EMA orphan designation EU/3/15/1521: Garsun
Active substance: artesunate
Designated 2015-07-28. Orphan designation status: Positive. EMA product number: EMEA/H/C/005718.
First published: 2015-08-18. Last updated: 2020-06-08.

Intended use: Treatment of malaria

Source: https://www.ema.europa.eu/en/medicines/human/orphan-designations/eu-3-15-1521